EMA orphan designation EU/3/14/1241
Active substance: Asp-Arg-Val-Tyr-Ile-His-Pro (Angiotensin 1-7); talfirastide
Designated 2014-02-19. Orphan designation status: Positive.
First published: 2014-04-01. Last updated: 2024-10-22.

Intended use: Treatment of Duchenne muscular dystrophy

Source: https://www.ema.europa.eu/en/medicines/human/orphan-designations/eu-3-14-1241